EMA orphan designation EU/3/23/2815
Active substance: allogeneic peripheral blood-derived haematopoietic stem and progenitor cells, regulatory T cells and conventional T cells
Designated 2023-07-25. Orphan designation status: Positive.
First published: 2024-02-01.

Intended use: Treatment in haematopoietic stem cell transplantation

Source: https://www.ema.europa.eu/en/medicines/human/orphan-designations/eu-3-23-2815